EMA orphan designation EU/3/09/721
Active substance: panobinostat
Designated 2010-02-02. Orphan designation status: Withdrawn.
First published: 2010-03-04. Last updated: 2015-02-11.

Intended use: Treatment of Hodgkin's lymphoma

Source: https://www.ema.europa.eu/en/medicines/human/orphan-designations/eu-3-09-721